EMA orphan designation EU/3/20/2282
Active substance: viltolarsen
Designated 2020-06-04. Orphan designation status: Positive.
First published: 2020-08-19. Last updated: 2023-06-01.

Intended use: Treatment of Duchenne muscular dystrophy

Source: https://www.ema.europa.eu/en/medicines/human/orphan-designations/eu-3-20-2282